EMA orphan designation EU/3/23/2801
Active substance: rhizobium rhizogenes; lipopolysaccharide
Designated 2023-07-25. Orphan designation status: Positive.
First published: 2024-02-01.

Intended use: Treatment of congenital diaphragmatic hernia

Source: https://www.ema.europa.eu/en/medicines/human/orphan-designations/eu-3-23-2801